EMA orphan designation EU/3/08/593
Active substance: N2'-Deacetyl-N2'-[4-methyl-4-(oxobuthyldithio)-1-oxopentyl]-maytansine-chimerized anti-CD138 IgG4 monoclonal antibody
Designated 2008-12-03. Orphan designation status: Withdrawn.
First published: 2009-06-29. Last updated: 2019-11-20.

Intended use: Treatment of multiple myeloma

Source: https://www.ema.europa.eu/en/medicines/human/orphan-designations/eu-3-08-593